EMA orphan designation EU/3/20/2358
Active substance: L-pyroglutamyl-L-asparaginyl-L-prolyl-D-tyrosyl-D-tryptophan amide
Designated 2020-11-13. Orphan designation status: Positive.
First published: 2021-02-15.

Intended use: Treatment of amyotrophic lateral sclerosis

Source: https://www.ema.europa.eu/en/medicines/human/orphan-designations/eu-3-20-2358